EMA orphan designation EU/3/15/1485
Active substance: sodium 2-hydroxylinoleate
Designated 2015-04-24. Orphan designation status: Positive.
First published: 2015-05-22. Last updated: 2015-05-22.

Intended use: Treatment of neuroblastoma

Source: https://www.ema.europa.eu/en/medicines/human/orphan-designations/eu-3-15-1485